EMA orphan designation EU/3/15/1568
Active substance: interferon alfa-n3
Designated 2015-11-11. Orphan designation status: Positive.
First published: 2016-01-05. Last updated: 2016-01-05.

Intended use: Treatment of Middle East respiratory syndrome

Source: https://www.ema.europa.eu/en/medicines/human/orphan-designations/eu-3-15-1568